EMA orphan designation EU/3/21/2479
Active substance: recombinant human ectonucleotide pyrophosphatase/phosphodiesterase 1 fused to the Fc fragment of IgG1
Designated 2021-07-19. Orphan designation status: Positive.
First published: 2021-11-16.

Intended use: Treatment of adenosine triphosphate binding cassette transporter protein subfamily C member 6 deficiency

Source: https://www.ema.europa.eu/en/medicines/human/orphan-designations/eu-3-21-2479